EMA orphan designation EU/3/14/1419
Active substance: tenofovir disoproxil fumarate
Designated 2015-01-15. Orphan designation status: Positive.
First published: 2015-03-05. Last updated: 2015-03-05.

Intended use: Treatment of Aicardi-Goutières syndrome

Source: https://www.ema.europa.eu/en/medicines/human/orphan-designations/eu-3-14-1419